EMA orphan designation EU/3/13/1226
Active substance: (6aS)-1,10-dimethoxy-6-methyl-5,6,6a,7-tetrahydro-4H-dibenzo[de,g]quinoline-2,9-diol
Designated 2014-01-16. Orphan designation status: Positive.
First published: 2014-03-21. Last updated: 2014-03-21.

Intended use: Treatment of dystrophic myotonia

Source: https://www.ema.europa.eu/en/medicines/human/orphan-designations/eu-3-13-1226